EMA orphan designation EU/3/06/360: Verkazia; Vekacia
Active substance: ciclosporin
Designated 2006-04-06. Orphan designation status: Positive. EMA product number: EMEA/H/C/004411; EMEA/H/C/000904.
First published: 2009-02-24. Last updated: 2018-07-12.

Intended use: Treatment of vernal keratoconjunctivitis

Source: https://www.ema.europa.eu/en/medicines/human/orphan-designations/eu-3-06-360